EMA orphan designation EU/3/14/1264
Active substance: Ibrutinib
Designated 2014-04-29. Orphan designation status: Withdrawn.
First published: 2014-06-04. Last updated: 2021-10-22.

Intended use: Treatment of lymphoplasmacytic lymphoma

Source: https://www.ema.europa.eu/en/medicines/human/orphan-designations/eu-3-14-1264